EMA orphan designation EU/3/15/1524
Active substance: triheptanoin
Designated 2015-07-28. Orphan designation status: Positive.
First published: 2015-08-18. Last updated: 2020-11-11.

Intended use: Treatment of long-chain 3-hydroxyacyl-coenzyme A dehydrogenase deficiency

Source: https://www.ema.europa.eu/en/medicines/human/orphan-designations/eu-3-15-1524